EMA orphan designation EU/3/06/426
Active substance: Fenretinide
Designated 2007-01-26. Orphan designation status: Withdrawn.
First published: 2008-07-29. Last updated: 2011-03-25.

Intended use: Treatment of primary malignant bone tumours

Source: https://www.ema.europa.eu/en/medicines/human/orphan-designations/eu-3-06-426